EMA orphan designation EU/3/18/2075
Active substance: gefinitib
Designated 2018-10-26. Orphan designation status: Positive.
First published: 2019-01-10. Last updated: 2022-11-11.

Intended use: Treatment of Fanconi anaemia type A

Source: https://www.ema.europa.eu/en/medicines/human/orphan-designations/eu-3-18-2075